EMA orphan designation EU/3/15/1587
Active substance: Combretastatin A1 diphosphate
Designated 2015-12-14. Orphan designation status: Positive.
First published: 2016-02-02. Last updated: 2022-09-28.

Intended use: Treatment of acute myeloid leukaemia

Source: https://www.ema.europa.eu/en/medicines/human/orphan-designations/eu-3-15-1587